EMA orphan designation EU/3/13/1164
Active substance: heterologous human adult liver-derived progenitor cells
Designated 2013-07-17. Orphan designation status: Positive.
First published: 2013-08-21. Last updated: 2024-10-15.

Intended use: Treatment of hyperargininaemia

Source: https://www.ema.europa.eu/en/medicines/human/orphan-designations/eu-3-13-1164